EMA orphan designation EU/3/14/1384
Designated 2014-12-16. Orphan designation status: Positive.
First published: 2015-02-26. Last updated: 2015-02-26.

Intended use: Treatment of WHIM syndrome

Source: https://www.ema.europa.eu/en/medicines/human/orphan-designations/eu-3-14-1384